EMA orphan designation EU/3/04/260: Lamzede
Active substance: recombinant human alpha-Mannosidase (velmanase alfa)
Designated 2005-01-26. Orphan designation status: Positive. EMA product number: EMEA/H/C/003922.
First published: 2005-07-01. Last updated: 2018-04-10.

Intended use: Treatment of alpha-mannosidosis

Source: https://www.ema.europa.eu/en/medicines/human/orphan-designations/eu-3-04-260